EMA orphan designation EU/3/13/1211
Active substance: allogeneic and autologous haptenised and irradiated cells and cell lysates derived from glioma
Designated 2014-01-16. Orphan designation status: Positive.
First published: 2014-01-22. Last updated: 2014-01-22.

Intended use: Treatment of glioma

Source: https://www.ema.europa.eu/en/medicines/human/orphan-designations/eu-3-13-1211